EMA orphan designation EU/3/08/535
Active substance: Humanised monoclonal antibody to the folate receptor alpha
Designated 2008-04-01. Orphan designation status: Positive.
First published: 2008-07-10. Last updated: 2020-08-11.

Intended use: Treatment of ovarian cancer

Source: https://www.ema.europa.eu/en/medicines/human/orphan-designations/eu-3-08-535